EMA orphan designation EU/3/22/2613
Active substance: streptococcus pyogenes, group A, type 3, strain Su, inactivated
Designated 2022-05-16. Orphan designation status: Positive.
First published: 2022-12-14. Last updated: 2023-04-04.

Intended use: Treatment of lymphatic malformations

Source: https://www.ema.europa.eu/en/medicines/human/orphan-designations/eu-3-22-2613